EMA orphan designation EU/3/20/2250: Elevidys
Active substance: adeno-associated virus serotype rh74 containing the human micro-dystrophin gene
Designated 2020-02-28. Orphan designation status: Positive. EMA product number: EMEA/H/C/005293.
First published: 2020-05-05. Last updated: 2020-10-22.

Intended use: Treatment of Duchenne muscular dystrophy

Source: https://www.ema.europa.eu/en/medicines/human/orphan-designations/eu-3-20-2250